EMA orphan designation EU/3/07/458
Active substance: Fampridine
Designated 2007-07-10. Orphan designation status: Withdrawn.
First published: 2008-01-17. Last updated: 2016-01-19.

Intended use: Treatment of Guillain-Barré syndrome

Source: https://www.ema.europa.eu/en/medicines/human/orphan-designations/eu-3-07-458